EMA orphan designation EU/3/09/717
Active substance: Ecopipam
Designated 2010-02-03. Orphan designation status: Positive.
First published: 2010-03-04. Last updated: 2010-03-04.

Intended use: Treatment of Lesch-Nyhan disease

Source: https://www.ema.europa.eu/en/medicines/human/orphan-designations/eu-3-09-717